EMA orphan designation EU/3/01/075
Active substance: Denileukin diftitox
Designated 2001-12-11. Orphan designation status: Positive.
First published: 2009-09-21. Last updated: 2022-01-19.

Intended use: Treatment of cutaneous T-cell lymphoma

Source: https://www.ema.europa.eu/en/medicines/human/orphan-designations/eu-3-01-075